EMA orphan designation EU/3/12/1079
Active substance: artesunate
Designated 2012-12-06. Orphan designation status: Positive.
First published: 2013-01-25. Last updated: 2013-01-25.

Intended use: Treatment of malaria

Source: https://www.ema.europa.eu/en/medicines/human/orphan-designations/eu-3-12-1079